EMA orphan designation EU/3/22/2626
Active substance: amitriptyline
Designated 2022-06-21. Orphan designation status: Withdrawn.
First published: 2022-09-15. Last updated: 2025-02-20.

Intended use: Treatment of erythromelalgia

Source: https://www.ema.europa.eu/en/medicines/human/orphan-designations/eu-3-22-2626